EMA orphan designation EU/3/18/1989
Active substance: (2S,4R)-1-(2-(3-acetyl-5-(2-methylpyrimidine-5-yl)-1H-indazol-1-yl)acetyl)-N-(6-bromopyridine-2-yl)-4-fluoropyrrolidine-2-carboxamide
Designated 2018-03-21. Orphan designation status: Withdrawn.
First published: 2018-05-08. Last updated: 2025-07-17.

Intended use: Treatment of C3 glomerulopathy

Source: https://www.ema.europa.eu/en/medicines/human/orphan-designations/eu-3-18-1989